EMA orphan designation EU/3/22/2610
Active substance: autologous T cells transduced with lentiviral vector containing a chimeric antigen receptor directed against CD123
Designated 2022-05-16. Orphan designation status: Positive.
First published: 2022-12-14.

Intended use: Treatment of blastic plasmacytoid dendritic cell neoplasm

Source: https://www.ema.europa.eu/en/medicines/human/orphan-designations/eu-3-22-2610